EMA orphan designation EU/3/16/1645
Active substance: cannabidiol
Designated 2016-04-28. Orphan designation status: Positive.
First published: 2016-05-30. Last updated: 2016-05-30.

Intended use: Prevention of graft-versus-host disease

Source: https://www.ema.europa.eu/en/medicines/human/orphan-designations/eu-3-16-1645